EMA orphan designation EU/3/24/3007
Active substance: Davunetide
Designated 2024-12-13. Orphan designation status: Positive.
First published: 2025-01-13.

Intended use: Treatment of activity-dependent neuroprotective protein (ADNP) syndrome

Source: https://www.ema.europa.eu/en/medicines/human/orphan-designations/eu-3-24-3007